EMA orphan designation EU/3/07/494
Active substance: lenalidomide
Designated 2007-11-19. Orphan designation status: Withdrawn.
First published: 2009-09-21. Last updated: 2020-01-13.

Intended use: Treatment of chronic lymphocytic leukaemia

Source: https://www.ema.europa.eu/en/medicines/human/orphan-designations/eu-3-07-494